EMA orphan designation EU/3/14/1284: Cresemba
Active substance: Isavuconazonium sulfate
Designated 2014-07-04. Orphan designation status: Positive. EMA product number: EMEA/H/C/002734.
First published: 2014-07-16. Last updated: 2019-05-21.

Intended use: Treatment of invasive aspergillosis

Source: https://www.ema.europa.eu/en/medicines/human/orphan-designations/eu-3-14-1284